EMA orphan designation EU/3/23/2770
Active substance: 5,5-dimethyl-3-[2-(7-methylspiro[2H-benzofuran-3,1'-cyclopropane]-4-yl)oxypyrimidin-5-yl]imidazolidine-2,4-dione
Designated 2023-03-20. Orphan designation status: Positive.
First published: 2024-01-31.

Intended use: Treatment of fragile X syndrome

Source: https://www.ema.europa.eu/en/medicines/human/orphan-designations/eu-3-23-2770